EMA orphan designation EU/3/04/203
Active substance: 5'-CTG CCA CGT TCT CCT GC-(2' methoxy)A-(2' methoxy)C-(2' methoxy)C-3'
Designated 2004-06-21. Orphan designation status: Withdrawn.
First published: 2005-09-06. Last updated: 2019-08-30.

Intended use: Treatment of myasthenia gravis

Source: https://www.ema.europa.eu/en/medicines/human/orphan-designations/eu-3-04-203